EMA orphan designation EU/3/03/145: Orathecin
Active substance: rubitecan
Designated 2003-06-10. Orphan designation status: Positive. EMA product number: EMEA/H/C/000608.
First published: 2003-06-23. Last updated: 2003-06-23.

Intended use: Treatment of pancreatic cancer

Source: https://www.ema.europa.eu/en/medicines/human/orphan-designations/eu-3-03-145